EMA orphan designation EU/3/19/2225
Active substance: replication-incompetent, non-integrating, herpes simplex virus 1 vector expressing the human transglutaminase-1 enzyme (Inetagugene geperpavec)
Designated 2019-11-13. Orphan designation status: Positive.
First published: 2020-02-13. Last updated: 2023-01-13.

Intended use: Treatment of autosomal recessive congenital ichthyosis

Source: https://www.ema.europa.eu/en/medicines/human/orphan-designations/eu-3-19-2225